EMA orphan designation EU/3/11/911
Active substance: recombinant human galactocerebrosidase
Designated 2011-09-27. Orphan designation status: Withdrawn.
First published: 2011-10-17. Last updated: 2020-08-12.

Intended use: Treatment of globoid cell leukodystrophy (Krabbe disease)

Source: https://www.ema.europa.eu/en/medicines/human/orphan-designations/eu-3-11-911